EMA orphan designation EU/3/20/2286
Active substance: autologous CD4+ and CD8+ T cells transduced with a lentiviral vector encoding an affinity enhanced T cell receptor specific to MAGE-A4
Designated 2020-06-04. Orphan designation status: Positive.
First published: 2020-08-19.

Intended use: Treatment of soft tissue sarcoma

Source: https://www.ema.europa.eu/en/medicines/human/orphan-designations/eu-3-20-2286